EMA orphan designation EU/3/19/2189
Active substance: gallium citrate
Designated 2019-08-21. Orphan designation status: Positive.
First published: 2020-01-14. Last updated: 2021-07-13.

Intended use: Treatment of cystic fibrosis

Source: https://www.ema.europa.eu/en/medicines/human/orphan-designations/eu-3-19-2189